EMA orphan designation EU/3/10/784
Active substance: recombinant porcine factor VIII (B-domain-deleted)
Designated 2010-09-20. Orphan designation status: Withdrawn.
First published: 2010-10-18. Last updated: 2016-01-19.

Intended use: Treatment of haemophilia A

Source: https://www.ema.europa.eu/en/medicines/human/orphan-designations/eu-3-10-784